EMA orphan designation EU/3/06/422
Active substance: paclitaxel
Designated 2006-12-17. Orphan designation status: Withdrawn.
First published: 2009-04-02. Last updated: 2018-12-05.

Intended use: Treatment of ovarian cancer

Source: https://www.ema.europa.eu/en/medicines/human/orphan-designations/eu-3-06-422